EMA orphan designation EU/3/03/133
Active substance: icatibant acetate
Designated 2003-02-18. Orphan designation status: Expired.
First published: 2003-04-09. Last updated: 2020-08-12.

Intended use: Treatment of angioedema

Source: https://www.ema.europa.eu/en/medicines/human/orphan-designations/eu-3-03-133